EMA orphan designation EU/3/15/1441
Active substance: 5-hydroxymethyl-2-furfural
Designated 2015-02-12. Orphan designation status: Withdrawn.
First published: 2015-04-09. Last updated: 2016-02-10.

Intended use: Treatment of sickle cell disease

Source: https://www.ema.europa.eu/en/medicines/human/orphan-designations/eu-3-15-1441